EMA orphan designation EU/3/15/1538
Active substance: fosfomycin disodium; tobramycin
Designated 2015-08-10. Orphan designation status: Positive.
First published: 2015-10-02. Last updated: 2015-10-02.

Intended use: Treatment of cystic fibrosis

Source: https://www.ema.europa.eu/en/medicines/human/orphan-designations/eu-3-15-1538